EMA orphan designation EU/3/01/041
Active substance: recombinant human alpha-1 antitrypsin
Designated 2001-05-30. Orphan designation status: Withdrawn.
First published: 2009-05-15. Last updated: 2009-05-15.

Intended use: Treatment of emphysema secondary to congenital alpha-1 antitrypsin deficiency

Source: https://www.ema.europa.eu/en/medicines/human/orphan-designations/eu-3-01-041